EMA orphan designation EU/3/13/1115
Active substance: 1-[(3R)-3-[4-amino-3-(4-phenoxyphenyl)-1H-pyrazolo[3,4 d]pyrimidin-1-yl]-1-piperidinyl]-2-propen-1-one (ibrutinib)
Designated 2013-03-12. Orphan designation status: Withdrawn.
First published: 2013-04-05. Last updated: 2021-10-22.

Intended use: Treatment of mantle cell lymphoma

Source: https://www.ema.europa.eu/en/medicines/human/orphan-designations/eu-3-13-1115